EMA orphan designation EU/3/24/2936
Active substance: Complement factor H
Designated 2024-06-28. Orphan designation status: Positive.
First published: 2024-08-22.

Intended use: Treatment of C3 glomerulopathy

Source: https://www.ema.europa.eu/en/medicines/human/orphan-designations/eu-3-24-2936